EMA orphan designation EU/3/08/579: Holoclar
Active substance: ex-vivo-expanded autologous human corneal epithelium-containing stem cells
Designated 2008-11-07. Orphan designation status: Positive. EMA product number: EMEA/H/C/002450.
First published: 2009-04-24. Last updated: 2025-03-07.

Intended use: Corneal lesions, with associated corneal (limbal) stem cell deficiency, due to ocular burns

Source: https://www.ema.europa.eu/en/medicines/human/orphan-designations/eu-3-08-579